EMA orphan designation EU/3/23/2810
Active substance: nomacopan
Designated 2023-07-25. Orphan designation status: Positive.
First published: 2024-02-01.

Intended use: Treatment in haematopoietic stem cell transplantation

Source: https://www.ema.europa.eu/en/medicines/human/orphan-designations/eu-3-23-2810